EMA orphan designation EU/3/16/1686
Active substance: recombinant humanised monoclonal IgG2 lambda antibody against human sclerostin
Designated 2016-06-27. Orphan designation status: Positive.
First published: 2016-07-26. Last updated: 2017-08-16.

Intended use: Treatment of osteogenesis imperfecta

Source: https://www.ema.europa.eu/en/medicines/human/orphan-designations/eu-3-16-1686